EMA orphan designation EU/3/25/3110
Active substance: emugrobart
Designated 2025-08-22. Orphan designation status: Positive.
First published: 2026-04-10.

Intended use: Treatment of spinal muscular atrophy

Source: https://www.ema.europa.eu/en/medicines/human/orphan-designations/eu-3-25-3110